EMA orphan designation EU/3/22/2587
Active substance: tropatepine hydrochloride
Designated 2022-03-16. Orphan designation status: Positive.
First published: 2022-06-16.

Intended use: Treatment of narcolepsy

Source: https://www.ema.europa.eu/en/medicines/human/orphan-designations/eu-3-22-2587